EMA orphan designation EU/3/02/092
Active substance: 4-(3,5-Bis(hydroxy-phenyl)-1,2,4) triazol-1-yl) benzoic acid
Designated 2002-03-13. Orphan designation status: Expired.
First published: 2007-02-28. Last updated: 2016-09-30.

Intended use: Treatment of chronic iron overload requiring chelation therapy

Source: https://www.ema.europa.eu/en/medicines/human/orphan-designations/eu-3-02-092